EMA orphan designation EU/3/25/3179
Active substance: lanthanum carbonate octahydrate
Designated 2026-01-09. Orphan designation status: Positive.
First published: 2026-04-10.

Intended use: Treatment of primary hyperoxaluria

Source: https://www.ema.europa.eu/en/medicines/human/orphan-designations/eu-3-25-3179